EMA orphan designation EU/3/17/1962
Active substance: Humanised Fc-engineered monoclonal antibody against CD19
Designated 2018-01-17. Orphan designation status: Positive.
First published: 2018-03-12. Last updated: 2018-03-12.

Intended use: Treatment of IgG4-related disease

Source: https://www.ema.europa.eu/en/medicines/human/orphan-designations/eu-3-17-1962